EMA orphan designation EU/3/21/2543
Active substance: copper (64Cu) chloride
Designated 2021-12-10. Orphan designation status: Positive.
First published: 2022-04-20.

Intended use: Treatment of glioma

Source: https://www.ema.europa.eu/en/medicines/human/orphan-designations/eu-3-21-2543